EMA orphan designation EU/3/20/2306
Active substance: retinol palmitate
Designated 2020-07-27. Orphan designation status: Positive.
First published: 2020-11-19.

Intended use: Prevention of bronchopulmonary dysplasia

Source: https://www.ema.europa.eu/en/medicines/human/orphan-designations/eu-3-20-2306